EMA orphan designation EU/3/16/1776
Active substance: ascorbic acid
Designated 2016-11-18. Orphan designation status: Positive.
First published: 2016-12-14. Last updated: 2016-12-14.

Intended use: treatment of facioscapulohumeral muscular dystrophy

Source: https://www.ema.europa.eu/en/medicines/human/orphan-designations/eu-3-16-1776